EMA orphan designation EU/3/21/2481
Active substance: Loncastuximab tesirine
Designated 2021-08-20. Orphan designation status: Withdrawn.
First published: 2022-02-25. Last updated: 2023-01-26.

Intended use: Treatment of diffuse large B-cell lymphoma

Source: https://www.ema.europa.eu/en/medicines/human/orphan-designations/eu-3-21-2481